EMA orphan designation EU/3/15/1551: Takhzyro
Active substance: recombinant human IgG1 kappa light chain monoclonal antibody targeting plasma kallikrein (lanadelumab)
Designated 2015-10-09. Orphan designation status: Positive. EMA product number: EMEA/H/C/004806.
First published: 2015-11-16. Last updated: 2022-10-04.

Intended use: Treatment of hereditary angioedema

Source: https://www.ema.europa.eu/en/medicines/human/orphan-designations/eu-3-15-1551